EMA orphan designation EU/3/03/134
Active substance: Iodine (123I) Serum Amyloid P
Designated 2003-02-14. Orphan designation status: Withdrawn.
First published: 2008-07-29. Last updated: 2016-08-10.

Intended use: Diagnosis of the extent of histologically proven amyloidosis

Source: https://www.ema.europa.eu/en/medicines/human/orphan-designations/eu-3-03-134